EMA orphan designation EU/3/10/838
Active substance: plitidepsin
Designated 2011-02-23. Orphan designation status: Withdrawn.
First published: 2011-03-07. Last updated: 2011-09-06.

Intended use: Treatment of post-essential thrombocythaemia myelofibrosis

Source: https://www.ema.europa.eu/en/medicines/human/orphan-designations/eu-3-10-838